EMA orphan designation EU/3/22/2596
Active substance: adeno-associated virus serotype 9 containing human MYBPC3 gene
Designated 2022-04-13. Orphan designation status: Positive.
First published: 2022-07-18.

Intended use: Treatment of Hypertrophic cardiomyopathy due to mutations in the MYBPC3 gene encoding cardiac myosin-binding protein C

Source: https://www.ema.europa.eu/en/medicines/human/orphan-designations/eu-3-22-2596